EMA orphan designation EU/3/01/036
Active substance: recombinant human C1-inhibitor
Designated 2001-05-11. Orphan designation status: Withdrawn.
First published: 2009-05-15. Last updated: 2010-11-18.

Intended use: Treatment of angioedema caused by C1 inhibitor deficiency

Source: https://www.ema.europa.eu/en/medicines/human/orphan-designations/eu-3-01-036